EMA orphan designation EU/3/06/417
Active substance: human interleukin-2 (glycosylated tetrasaccharide, glycosylated trisaccharide and non-glycosylated)
Designated 2006-10-27. Orphan designation status: Positive.
First published: 2008-07-29. Last updated: 2016-04-11.

Intended use: Treatment of renal-cell carcinoma

Source: https://www.ema.europa.eu/en/medicines/human/orphan-designations/eu-3-06-417